EMA orphan designation EU/3/20/2376
Active substance: 4-[(3S)-3-aminopyrrolidin-1-yl]-6-cyano-5-(3,5-difluorophenyl)-N-[(2S)-1,1,1-trifluoropropan-2-yl]pyridine-3-carboxamide
Designated 2020-12-09. Orphan designation status: Withdrawn.
First published: 2021-05-27. Last updated: 2026-05-18.

Intended use: Treatment of congenital hyperinsulinism

Source: https://www.ema.europa.eu/en/medicines/human/orphan-designations/eu-3-20-2376